EMA orphan designation EU/3/16/1746
Active substance: carbamazepine
Designated 2016-10-14. Orphan designation status: Positive.
First published: 2016-11-15. Last updated: 2016-11-15.

Intended use: Treatment of metaphyseal chondrodysplasia, Schmid type

Source: https://www.ema.europa.eu/en/medicines/human/orphan-designations/eu-3-16-1746